EMA orphan designation EU/3/16/1805
Active substance: propranolol
Designated 2016-12-12. Orphan designation status: Positive.
First published: 2017-01-09. Last updated: 2017-01-09.

Intended use: Treatment of soft tissue sarcoma

Source: https://www.ema.europa.eu/en/medicines/human/orphan-designations/eu-3-16-1805